EMA orphan designation EU/3/22/2694
Active substance: autologous T cells transduced with a lentiviral vector expressing a chimeric antigen receptor against CLL-1
Designated 2022-10-11. Orphan designation status: Positive.
First published: 2023-01-24.

Intended use: Treatment of acute myeloid leukaemia

Source: https://www.ema.europa.eu/en/medicines/human/orphan-designations/eu-3-22-2694